EMA orphan designation EU/3/25/3081
Active substance: 2-[[Hydroxy[(R)-2-[((5Z,8Z,11Z,14Z)-eicosa-5,8,11,14-tetraenoyl)oxy]-3-(octadecyloxy)propoxy]phosphoryl]oxy]ethan-1-amine
Designated 2025-06-20. Orphan designation status: Positive.
First published: 2025-07-25.

Intended use: Treatment of Rett syndrome

Source: https://www.ema.europa.eu/en/medicines/human/orphan-designations/eu-3-25-3081